EMA orphan designation EU/3/18/1992: Vyvgart
Active substance: efgartigimod alfa
Orphan designation status: Positive. EMA product number: EMEA/H/C/005849.
First published: 2018-05-17. Last updated: 2022-09-12.

Intended use: Treatment of myasthenia gravis

Source: https://www.ema.europa.eu/en/medicines/human/orphan-designations/eu-3-18-1992